EMA orphan designation EU/3/06/373
Active substance: Mecasermin
Designated 2006-05-22. Orphan designation status: Expired.
First published: 2009-04-24. Last updated: 2017-10-03.

Intended use: Treatment of primary insulin-like growth factor-1 deficiency due to molecular or genetic defects

Source: https://www.ema.europa.eu/en/medicines/human/orphan-designations/eu-3-06-373